EMA orphan designation EU/3/09/660
Active substance: trabedersen
Designated 2009-07-24. Orphan designation status: Positive.
First published: 2009-09-11. Last updated: 2022-03-18.

Intended use: Treatment of pancreatic cancer

Source: https://www.ema.europa.eu/en/medicines/human/orphan-designations/eu-3-09-660